EMA orphan designation EU/3/14/1255
Active substance: volasertib
Designated 2014-03-26. Orphan designation status: Positive.
First published: 2014-05-06. Last updated: 2014-05-06.

Intended use: Treatment of acute myeloid leukaemia

Source: https://www.ema.europa.eu/en/medicines/human/orphan-designations/eu-3-14-1255